EMA orphan designation EU/3/17/1907
Active substance: recombinant fragment of human surfactant protein-D
Designated 2017-08-23. Orphan designation status: Positive.
First published: 2017-10-17. Last updated: 2019-08-19.

Intended use: Prevention of bronchopulmonary dysplasia

Source: https://www.ema.europa.eu/en/medicines/human/orphan-designations/eu-3-17-1907